EMA orphan designation EU/3/11/862
Active substance: apomorphine hydrochloride
Designated 2011-05-13. Orphan designation status: Positive.
First published: 2011-05-27. Last updated: 2011-05-27.

Intended use: Treatment of moderate and severe traumatic brain injury

Source: https://www.ema.europa.eu/en/medicines/human/orphan-designations/eu-3-11-862